EMA orphan designation EU/3/13/1219: Fintepla
Active substance: fenfluramine hydrochloride
Designated 2013-12-18. Orphan designation status: Positive. EMA product number: EMEA/H/C/003933.
First published: 2014-01-23. Last updated: 2023-04-25.

Intended use: Treatment of Dravet syndrome

Source: https://www.ema.europa.eu/en/medicines/human/orphan-designations/eu-3-13-1219